EMA orphan designation EU/3/24/2946
Active substance: urokinase, catalytic domain, fused with a single-chain antibody against von Willebrand factor
Designated 2024-07-25. Orphan designation status: Positive.
First published: 2024-09-13.

Intended use: Treatment of thrombotic thrombocytopenic purpura

Source: https://www.ema.europa.eu/en/medicines/human/orphan-designations/eu-3-24-2946